EMA orphan designation EU/3/13/1116
Active substance: Mepolizumab
Designated 2013-03-12. Orphan designation status: Withdrawn.
First published: 2013-04-05. Last updated: 2020-10-23.

Intended use: Treatment of Churg-Strauss syndrome

Source: https://www.ema.europa.eu/en/medicines/human/orphan-designations/eu-3-13-1116